EMA orphan designation EU/3/20/2368
Active substance: human interleukin 12 fused with immunoglobulin G4 C-terminal Fc fragment
Designated 2020-12-09. Orphan designation status: Positive.
First published: 2021-05-19.

Intended use: Treatment of glioma

Source: https://www.ema.europa.eu/en/medicines/human/orphan-designations/eu-3-20-2368